EMA orphan designation EU/3/20/2313
Active substance: 2-(2-(18F)fluoropyridin-4-yl)-9H-pyrrolo[2,3-b:4,5-c']dipyridine
Designated 2020-08-21. Orphan designation status: Positive.
First published: 2020-11-25.

Intended use: Diagnosis of progressive supranuclear palsy

Source: https://www.ema.europa.eu/en/medicines/human/orphan-designations/eu-3-20-2313